EMA orphan designation EU/3/07/463
Active substance: pyridoxalated hemoglobin polyoxyethylene
Designated 2007-08-02. Orphan designation status: Positive.
First published: 2008-07-29. Last updated: 2008-07-29.

Intended use: Treatment of cardiogenic shock

Source: https://www.ema.europa.eu/en/medicines/human/orphan-designations/eu-3-07-463